EMA orphan designation EU/3/18/2039
Active substance: recombinant adeno-associated viral vector serotype 9 containing human iduronidase gene
Designated 2018-06-27. Orphan designation status: Positive.
First published: 2018-08-22. Last updated: 2018-08-22.

Intended use: Treatment of mucopolysaccharidosis type I

Source: https://www.ema.europa.eu/en/medicines/human/orphan-designations/eu-3-18-2039